EMA orphan designation EU/3/25/3077
Active substance: Humanised IgG1 monoclonal antibody against adrenocorticotropic hormone
Designated 2025-06-20. Orphan designation status: Positive.
First published: 2025-07-25.

Intended use: Treatment of congenital adrenal hyperplasia

Source: https://www.ema.europa.eu/en/medicines/human/orphan-designations/eu-3-25-3077